EMA orphan designation EU/3/18/2038
Active substance: palovarotene
Designated 2018-06-27. Orphan designation status: Withdrawn.
First published: 2018-08-21. Last updated: 2021-10-19.

Intended use: Treatment of multiple osteochondromas

Source: https://www.ema.europa.eu/en/medicines/human/orphan-designations/eu-3-18-2038